EMA orphan designation EU/3/18/1974
Active substance: 6-{[(1R,2S)-2-aminocyclohexyl]amino}-7-fluoro-4-(1-methyl-1H-pyrazol-4-yl)-1,2-dihydro-3H-pyrrolo[3,4-c]pyridin-3-one monocitrate (also known as mivavotinib)
Designated 2018-02-22. Orphan designation status: Positive.
First published: 2018-04-10. Last updated: 2022-05-16.

Intended use: Treatment of acute myeloid leukaemia

Source: https://www.ema.europa.eu/en/medicines/human/orphan-designations/eu-3-18-1974